EMA orphan designation EU/3/22/2689
Active substance: (7S)-8,8-dimethyl-7-{[(2E)-3-phenyl-2-propen-1-yl]oxy}-7,8-dihydro-2H,6H-pyrano[3,2-g]chromen-2-one
Designated 2022-08-10. Orphan designation status: Positive.
First published: 2022-12-15.

Intended use: Treatment of Hutchinson-Gilford progeria syndrome

Source: https://www.ema.europa.eu/en/medicines/human/orphan-designations/eu-3-22-2689